EMA orphan designation EU/3/15/1468
Active substance: adeno-associated viral vector serotype 9 containing the human glucocerebrosidase gene
Designated 2015-03-19. Orphan designation status: Withdrawn.
First published: 2015-05-11. Last updated: 2017-07-17.

Intended use: Treatment of Gaucher disease

Source: https://www.ema.europa.eu/en/medicines/human/orphan-designations/eu-3-15-1468